EMA orphan designation EU/3/15/1457: Turalio
Active substance: [5-(5-chloro-1H-pyrrolo[2,3-b]pyridin-3-ylmethyl)-pyridin-2-yl]-(6-trifluoromethyl-pyridin-3-ylmethyl)-amine hydrochloride
Designated 2015-03-19. Orphan designation status: Withdrawn. EMA product number: EMEA/H/C/004832.
First published: 2015-05-11. Last updated: 2022-02-02.

Intended use: Treatment of tenosynovial giant cell tumour, localised and diffuse type

Source: https://www.ema.europa.eu/en/medicines/human/orphan-designations/eu-3-15-1457